EMA orphan designation EU/3/16/1627: Ebvallo
Active substance: allogeneic Epstein-Barr virus specific cytotoxic T lymphocytes (Tabelecleucel)
Designated 2016-03-21. Orphan designation status: Positive. EMA product number: EMEA/H/C/004577.
First published: 2016-05-03. Last updated: 2023-01-30.

Intended use: Treatment of post-transplantation lymphoproliferative disorders

Source: https://www.ema.europa.eu/en/medicines/human/orphan-designations/eu-3-16-1627